EMA orphan designation EU/3/18/2105
Active substance: 6,8-bis(benzylthio)octanoic acid
Designated 2018-12-14. Orphan designation status: Positive.
First published: 2019-03-12.

Intended use: Treatment of pancreatic cancer

Source: https://www.ema.europa.eu/en/medicines/human/orphan-designations/eu-3-18-2105